EMA orphan designation EU/3/08/568
Active substance: N'-(5-chloro-2-hydroxy-3-methylbenzylidene)-2,4-dihydroxybenzhydrazide
Designated 2008-09-22. Orphan designation status: Positive.
First published: 2009-04-24. Last updated: 2011-04-06.

Intended use: Treatment of partial deep dermal and full-thickness burns

Source: https://www.ema.europa.eu/en/medicines/human/orphan-designations/eu-3-08-568